EMA orphan designation EU/3/17/1829
Active substance: 26 base synthetic single-stranded fully phosphorothioated 2'-O-methyl-RNA and DNA mixmer oligonucleotide-based compound
Designated 2017-02-27. Orphan designation status: Positive.
First published: 2017-03-31. Last updated: 2023-06-09.

Intended use: Treatment of Dravet syndrome

Source: https://www.ema.europa.eu/en/medicines/human/orphan-designations/eu-3-17-1829